EMA orphan designation EU/3/23/2845
Active substance: adeno-associated viral vector serotype 9 containing the human PLA2G6 gene
Designated 2023-12-10. Orphan designation status: Positive.
First published: 2024-01-24.

Intended use: Treatment of infantile neuroaxonal dystrophy

Source: https://www.ema.europa.eu/en/medicines/human/orphan-designations/eu-3-23-2845